EMA orphan designation EU/3/19/2192: Imcivree
Active substance: setmelanotide
Designated 2019-08-21. Orphan designation status: Positive. EMA product number: EMEA/H/C/005089.
First published: 2020-01-14. Last updated: 2022-09-30.

Intended use: Treatment of Bardet-Biedl syndrome

Source: https://www.ema.europa.eu/en/medicines/human/orphan-designations/eu-3-19-2192